EMA orphan designation EU/3/09/664
Active substance: allogeneic ex-vivo-expanded umbilical-cord blood cells
Designated 2009-10-08. Orphan designation status: Withdrawn.
First published: 2009-10-20. Last updated: 2015-05-08.

Intended use: Treatment of myelodysplastic syndromes

Source: https://www.ema.europa.eu/en/medicines/human/orphan-designations/eu-3-09-664